EMA orphan designation EU/3/22/2674
Active substance: Lithium carbonate
Designated 2022-08-10. Orphan designation status: Positive.
First published: 2022-12-15.

Intended use: Treatment of familial adenomatous polyposis (FAP)

Source: https://www.ema.europa.eu/en/medicines/human/orphan-designations/eu-3-22-2674